EMA orphan designation EU/3/21/2536
Active substance: vatiquinone
Designated 2021-12-10. Orphan designation status: Withdrawn.
First published: 2022-04-13. Last updated: 2026-05-13.

Intended use: Treatment of Alpers-Huttenlocher syndrome

Source: https://www.ema.europa.eu/en/medicines/human/orphan-designations/eu-3-21-2536